EMA orphan designation EU/3/16/1747
Active substance: chemically modified human recombinant sulfamidase
Designated 2016-10-14. Orphan designation status: Positive.
First published: 2016-11-15. Last updated: 2016-11-15.

Intended use: Treatment of mucopolysaccharidosis, type IIIA (Sanfilippo A syndrome)

Source: https://www.ema.europa.eu/en/medicines/human/orphan-designations/eu-3-16-1747